EMA orphan designation EU/3/21/2488
Active substance: autologous CD34+ cells transfected with a lentiviral vector containing codon optimised RPS19 gene
Designated 2021-08-20. Orphan designation status: Positive.
First published: 2022-02-25. Last updated: 2022-06-08.

Intended use: Treatment of Diamond-Blackfan anaemia

Source: https://www.ema.europa.eu/en/medicines/human/orphan-designations/eu-3-21-2488